EMA orphan designation EU/3/17/1920
Active substance: cannabidiol
Designated 2017-10-16. Orphan designation status: Positive.
First published: 2018-01-08. Last updated: 2023-06-15.

Intended use: Treatment of West syndrome

Source: https://www.ema.europa.eu/en/medicines/human/orphan-designations/eu-3-17-1920